EMA orphan designation EU/3/23/2847
Active substance: human IgG1 (296-cysteine,301-glycine,306-cysteine) monoclonal antibody against TREM2
Designated 2023-10-13. Orphan designation status: Withdrawn.
First published: 2024-02-01. Last updated: 2025-09-04.

Intended use: Treatment of CSF1R-related leukoencephalopathy

Source: https://www.ema.europa.eu/en/medicines/human/orphan-designations/eu-3-23-2847